EMA orphan designation EU/3/22/2699
Active substance: aglatimagene besadenovec
Designated 2022-10-11. Orphan designation status: Positive.
First published: 2023-01-24. Last updated: 2025-04-09.

Intended use: Treatment of glioma

Source: https://www.ema.europa.eu/en/medicines/human/orphan-designations/eu-3-22-2699